EMA orphan designation EU/3/06/357
Active substance: 2'-O-methyl-phosphorothioate oligonucleotide
Designated 2006-02-16. Orphan designation status: Withdrawn.
First published: 2009-10-15. Last updated: 2009-10-15.

Intended use: Treatment of Duchenne muscular dystrophy

Source: https://www.ema.europa.eu/en/medicines/human/orphan-designations/eu-3-06-357